EMA orphan designation EU/3/07/522
Active substance: (Manganese, dichloro [(4aR, 13aR, 17aR, 21aR)-1, 2, 3, 4, 4a, 5, 6, 12, 13, 13a, 14, 15, 16, 17, 17a, 18, 19, 20, 21, 21a-eicosahydro-11, 7-nitrilo-7H-dibenzo[ b,h] [1,4,7,10] tetraazacycloheptadecine-κN5, κN13, κN18, κN21, κN22]-) (misopasem manganese)
Designated 2008-01-31. Orphan designation status: Positive.
First published: 2008-07-10. Last updated: 2019-05-17.

Intended use: Prevention of oral mucositis in head and neck cancer patients undergoing radiation therapy

Source: https://www.ema.europa.eu/en/medicines/human/orphan-designations/eu-3-07-522